EMA orphan designation EU/3/07/446: Libmeldy
Active substance: autologous CD34+ cells transfected with lentiviral vector containing the human arylsulfatase A cDNA
Designated 2007-04-13. Orphan designation status: Positive. EMA product number: EMEA/H/C/005321.
First published: 2008-07-29. Last updated: 2022-07-14.

Intended use: treatment of metachromatic leukodystrophy

Source: https://www.ema.europa.eu/en/medicines/human/orphan-designations/eu-3-07-446